EMA orphan designation EU/3/01/068
Active substance: thalidomide
Designated 2001-12-19. Orphan designation status: Withdrawn.
First published: 2009-10-15. Last updated: 2009-10-15.

Intended use: Treatment of multiple myeloma

Source: https://www.ema.europa.eu/en/medicines/human/orphan-designations/eu-3-01-068